EMA orphan designation EU/3/06/428
Active substance: forodesine hydrochloride
Designated 2007-01-29. Orphan designation status: Positive.
First published: 2007-12-13. Last updated: 2019-05-21.

Intended use: Treatment of cutaneous T-cell lymphoma

Source: https://www.ema.europa.eu/en/medicines/human/orphan-designations/eu-3-06-428